EMA orphan designation EU/3/22/2667
Active substance: liraglutide
Designated 2022-07-18. Orphan designation status: Positive.
First published: 2023-01-23.

Intended use: Treatment of Wolfram syndrome

Source: https://www.ema.europa.eu/en/medicines/human/orphan-designations/eu-3-22-2667